EMA orphan designation EU/3/17/1861
Active substance: (S)-8-{2-amino-6-[1-(5-chloro-biphenyl-2-yl)-(R)-2,2,2-trifluoro-ethoxy]-pyrimidin-4-yl}-2,8-diaza-spiro[4.5]decane-3-carboxylic acid ethyl ester
Designated 2017-04-20. Orphan designation status: Positive.
First published: 2017-05-15. Last updated: 2024-04-11.

Intended use: Treatment of pulmonary arterial hypertension

Source: https://www.ema.europa.eu/en/medicines/human/orphan-designations/eu-3-17-1861